EMA orphan designation EU/3/18/2010
Active substance: branaplam
Designated 2018-04-16. Orphan designation status: Withdrawn.
First published: 2018-05-17. Last updated: 2024-04-11.

Intended use: Treatment of spinal muscular atrophy

Source: https://www.ema.europa.eu/en/medicines/human/orphan-designations/eu-3-18-2010